EMA orphan designation EU/3/22/2710
Active substance: Delpazolid
Designated 2022-11-10. Orphan designation status: Positive.
First published: 2023-01-23.

Intended use: Treatment of tuberculosis

Source: https://www.ema.europa.eu/en/medicines/human/orphan-designations/eu-3-22-2710